EMA orphan designation EU/3/09/705
Active substance: human monoclonal antibody against Pseudomonas aeruginosa IATS-O1
Designated 2010-01-28. Orphan designation status: Withdrawn.
First published: 2010-02-25. Last updated: 2021-02-03.

Intended use: Treatment of pneumonia caused by serotype O1 Pseudomonas aeruginosa

Source: https://www.ema.europa.eu/en/medicines/human/orphan-designations/eu-3-09-705